EMA orphan designation EU/3/21/2496
Active substance: autologous CD34+ cell enriched population containing haematopoietic stem and progenitor cells transduced ex vivo with a lentiviral vector encoding the human ADA2 gene
Designated 2021-08-20. Orphan designation status: Positive.
First published: 2022-03-04. Last updated: 2022-11-07.

Intended use: Treatment of adenosine deaminase 2 deficiency (DADA2)

Source: https://www.ema.europa.eu/en/medicines/human/orphan-designations/eu-3-21-2496